EMA orphan designation EU/3/13/1180
Active substance: apremilast
Designated 2013-08-05. Orphan designation status: Withdrawn.
First published: 2013-09-13. Last updated: 2017-05-17.

Intended use: Treatment of Behçet's Disease

Source: https://www.ema.europa.eu/en/medicines/human/orphan-designations/eu-3-13-1180